EMA orphan designation EU/3/12/1018
Active substance: recombinant adeno-associated viral vector containing human acid alfa-glucosidase-gene
Designated 2012-07-04. Orphan designation status: Positive.
First published: 2012-07-24. Last updated: 2019-06-18.

Intended use: Treatment of glycogen storage disease type II (Pompe's disease)

Source: https://www.ema.europa.eu/en/medicines/human/orphan-designations/eu-3-12-1018